EMA orphan designation EU/3/17/1971
Active substance: vatiquinone
Designated 2018-01-17. Orphan designation status: Withdrawn.
First published: 2018-03-12. Last updated: 2026-05-13.

Intended use: Treatment of RARS2 syndrome

Source: https://www.ema.europa.eu/en/medicines/human/orphan-designations/eu-3-17-1971